EMA orphan designation EU/3/22/2644
Active substance: Fosmanogepix
Designated 2022-07-18. Orphan designation status: Positive.
First published: 2023-01-23. Last updated: 2024-10-02.

Intended use: Treatment of invasive candidiasis

Source: https://www.ema.europa.eu/en/medicines/human/orphan-designations/eu-3-22-2644